EMA orphan designation EU/3/23/2760
Active substance: isotretinoin
Designated 2023-02-15. Orphan designation status: Withdrawn.
First published: 2024-01-24. Last updated: 2025-01-15.

Intended use: Treatment of recessive X-linked ichthyosis

Source: https://www.ema.europa.eu/en/medicines/human/orphan-designations/eu-3-23-2760